EMA orphan designation EU/3/03/182
Active substance: 5-Methyl-pyridine-2-sulfonic acid{6-(2-hydroxy-ethoxy)-5-(2-methoxy-phenoxy)-2-[2-(1H-tetrazol-5-yl)-pyridin-4-yl]-pyrimidin-4-yl}-amide sodium salt
Designated 2003-12-12. Orphan designation status: Withdrawn.
First published: 2005-12-12. Last updated: 2026-05-26.

Intended use: Treatment of aneurysmal subarachnoid haemorrhage

Source: https://www.ema.europa.eu/en/medicines/human/orphan-designations/eu-3-03-182